EMA orphan designation EU/3/23/2768: Fintepla
Active substance: fenfluramine hydrochloride
Designated 2023-03-20. Orphan designation status: Positive. EMA product number: EMEA/H/C/003933.
First published: 2024-01-31.

Intended use: Treatment of CDKL5 deficiency disorder

Source: https://www.ema.europa.eu/en/medicines/human/orphan-designations/eu-3-23-2768